EMA orphan designation EU/3/20/2325
Active substance: venglustat
Designated 2020-08-21. Orphan designation status: Positive.
First published: 2020-11-25. Last updated: 2023-07-18.

Intended use: Treatment of GM2 gangliosidosis

Source: https://www.ema.europa.eu/en/medicines/human/orphan-designations/eu-3-20-2325